EMA orphan designation EU/3/13/1200
Active substance: sorafenib tosilate
Designated 2013-11-13. Orphan designation status: Expired.
First published: 2013-12-02. Last updated: 2024-06-07.

Intended use: Treatment of papillary thyroid cancer

Source: https://www.ema.europa.eu/en/medicines/human/orphan-designations/eu-3-13-1200